EMA orphan designation EU/3/19/2194
Active substance: 4-(2-chloro-4-methoxy-5-methylphenyl)-N-[(1S)-2-cyclopropyl-1-(3-fluoro-4-methylphenyl)ethyl]-5-methyl-N-(2-propynyl)-1,3-thiazol-2-amine
Designated 2019-08-21. Orphan designation status: Positive.
First published: 2020-01-14.

Intended use: Treatment of congenital adrenal hyperplasia

Source: https://www.ema.europa.eu/en/medicines/human/orphan-designations/eu-3-19-2194